EMA orphan designation EU/3/10/833
Active substance: doxorubicin hydrochloride
Designated 2011-02-23. Orphan designation status: Positive.
First published: 2011-03-04. Last updated: 2019-01-22.

Intended use: Treatment of hepatocellular carcinoma

Source: https://www.ema.europa.eu/en/medicines/human/orphan-designations/eu-3-10-833